EMA orphan designation EU/3/21/2553
Active substance: troriluzole hydrochloride
Designated 2021-12-10. Orphan designation status: Positive.
First published: 2022-05-05. Last updated: 2023-05-08.

Intended use: Treatment of spinocerebellar ataxia

Source: https://www.ema.europa.eu/en/medicines/human/orphan-designations/eu-3-21-2553